EMA orphan designation EU/3/21/2570
Active substance: sirolimus
Designated 2022-01-14. Orphan designation status: Positive.
First published: 2022-06-15.

Intended use: Treatment of bronchiolitis obliterans syndrome (BOS)

Source: https://www.ema.europa.eu/en/medicines/human/orphan-designations/eu-3-21-2570